EMA orphan designation EU/3/21/2537
Active substance: retinol palmitate
Designated 2021-12-10. Orphan designation status: Positive.
First published: 2022-04-13.

Intended use: Prevention of bronchopulmonary dysplasia

Source: https://www.ema.europa.eu/en/medicines/human/orphan-designations/eu-3-21-2537